EMA orphan designation EU/3/24/2909
Active substance: autologous adipose-derived stem cells
Designated 2024-04-16. Orphan designation status: Positive.
First published: 2024-07-26.

Intended use: Treatment of spinal cord injury

Source: https://www.ema.europa.eu/en/medicines/human/orphan-designations/eu-3-24-2909